EMA orphan designation EU/3/01/059
Active substance: dexrazoxane
Designated 2001-09-19. Orphan designation status: Expired.
First published: 2009-06-15. Last updated: 2016-09-30.

Intended use: Treatment of anthracycline extravasations

Source: https://www.ema.europa.eu/en/medicines/human/orphan-designations/eu-3-01-059